EMA orphan designation EU/3/16/1646
Active substance: 4-hydroxyandrostenedione; serenoa serrulata; alpha lipoic acid
Designated 2016-04-28. Orphan designation status: Positive.
First published: 2016-05-31. Last updated: 2021-09-02.

Intended use: Treatment of multiple symmetric lipomatosis

Source: https://www.ema.europa.eu/en/medicines/human/orphan-designations/eu-3-16-1646